EMA orphan designation EU/3/13/1176
Active substance: human allogeneic bone-marrow-derived osteoblastic-like cells
Designated 2013-08-05. Orphan designation status: Positive.
First published: 2013-09-13. Last updated: 2022-12-22.

Intended use: Treatment of non-traumatic osteonecrosis

Source: https://www.ema.europa.eu/en/medicines/human/orphan-designations/eu-3-13-1176